EMA orphan designation EU/3/25/3108
Active substance: cladribine
Designated 2025-08-22. Orphan designation status: Positive.
First published: 2026-04-10.

Intended use: Treatment of acute myeloid leukaemia

Source: https://www.ema.europa.eu/en/medicines/human/orphan-designations/eu-3-25-3108